EMA orphan designation EU/3/06/400
Active substance: Metastable technetium 99 [99mTc] demogastrin 2
Designated 2006-08-28. Orphan designation status: Positive.
First published: 2009-04-24. Last updated: 2011-04-12.

Intended use: Diagnosis of medullary thyroid carcinoma

Source: https://www.ema.europa.eu/en/medicines/human/orphan-designations/eu-3-06-400